EMA orphan designation EU/3/03/158
Active substance: recombinant human arylsulfatase A
Designated 2003-07-09. Orphan designation status: Withdrawn.
First published: 2008-08-18. Last updated: 2011-11-18.

Intended use: treatment of metachromatic leukodystrophy

Source: https://www.ema.europa.eu/en/medicines/human/orphan-designations/eu-3-03-158